EMA orphan designation EU/3/17/1906
Active substance: recombinant adeno-associated viral vector serotype 5 carrying the gene for the human frataxin protein
Designated 2017-08-23. Orphan designation status: Positive.
First published: 2017-10-17. Last updated: 2018-12-18.

Intended use: Treatment of Friedreich's ataxia

Source: https://www.ema.europa.eu/en/medicines/human/orphan-designations/eu-3-17-1906